EMA orphan designation EU/3/23/2852
Active substance: rucosopasem manganese
Designated 2023-11-08. Orphan designation status: Positive.
First published: 2024-02-01.

Intended use: Treatment of pancreatic cancer

Source: https://www.ema.europa.eu/en/medicines/human/orphan-designations/eu-3-23-2852